EMA orphan designation EU/3/07/520
Active substance: Human-papilloma-virus-type-16 E6; E7 synthetic long peptides
Designated 2007-12-20. Orphan designation status: Positive.
First published: 2009-09-21. Last updated: 2013-06-26.

Intended use: Treatment of epithelial neoplasia of the vulva positive for human papilloma virus

Source: https://www.ema.europa.eu/en/medicines/human/orphan-designations/eu-3-07-520